EMA orphan designation EU/3/10/731
Active substance: bafetinib
Designated 2010-06-10. Orphan designation status: Withdrawn.
First published: 2010-06-24. Last updated: 2013-07-31.

Intended use: Treatment of chronic myeloid leukaemia

Source: https://www.ema.europa.eu/en/medicines/human/orphan-designations/eu-3-10-731